EMA orphan designation EU/3/06/412
Active substance: 4,7,10,13,16,19-docosahexaenoic acid
Designated 2006-11-04. Orphan designation status: Positive.
First published: 2009-04-02. Last updated: 2023-06-05.

Intended use: Treatment of retinitis pigmentosa

Source: https://www.ema.europa.eu/en/medicines/human/orphan-designations/eu-3-06-412